EMA orphan designation EU/3/19/2241
Active substance: adeno-associated virus serotype 2/6 encoding human alpha-galactosidase A cDNA
Designated 2020-01-09. Orphan designation status: Positive.
First published: 2020-05-19. Last updated: 2021-08-04.

Intended use: Treatment of Fabry disease

Source: https://www.ema.europa.eu/en/medicines/human/orphan-designations/eu-3-19-2241